EMA orphan designation EU/3/07/436
Active substance: eptacog alfa (activated)
Designated 2007-03-20. Orphan designation status: Withdrawn.
First published: 2007-12-04. Last updated: 2011-09-06.

Intended use: Treatment of post-neonatal intracerebral haemorrhage

Source: https://www.ema.europa.eu/en/medicines/human/orphan-designations/eu-3-07-436